EMA orphan designation EU/3/20/2253
Active substance: autologous skin equivalent graft composed of keratinocytes and fibroblasts genetically corrected by CRISPR/Cas9-mediated excision of mutation-carrying COL7A1 exon 80
Designated 2020-02-28. Orphan designation status: Positive.
First published: 2020-05-06. Last updated: 2022-11-11.

Intended use: Treatment of epidermolysis bullosa

Source: https://www.ema.europa.eu/en/medicines/human/orphan-designations/eu-3-20-2253